EMA orphan designation EU/3/04/188
Active substance: N-3[[4-(aminoiminomethyl)benzoyl]amino]propyl]-1-[[2,4—dichloro-3-[[2,4-dimethyl-8-quinolinyl)oxy]methyl]phenyl]sulphonyl]-(2S)-2-pyrrolidinecarboxamide, di(methanesulfonate)
Orphan designation status: Positive.
First published: 2007-02-23. Last updated: 2007-02-23.

Intended use: Treatment of moderate and severe traumatic brain injury

Source: https://www.ema.europa.eu/en/medicines/human/orphan-designations/eu-3-04-188